EMA orphan designation EU/3/04/216: Surfaxin
Active substance: sinapultide; Dipalmitoylphosphatidylcholine; palmitoyloleoyl phosphatidylglycerol; palmitic acid
Designated 2004-07-29. Orphan designation status: Positive. EMA product number: EMEA/H/C/000625.
First published: 2009-09-23. Last updated: 2019-05-22.

Intended use: Respiratory distress syndrome in premature neonates of less than 32 weeks of gestational age

Source: https://www.ema.europa.eu/en/medicines/human/orphan-designations/eu-3-04-216